EMA orphan designation EU/3/09/638
Active substance: Humanised IgG4 monoclonal antibody to the human toll-like receptor type 2
Designated 2009-05-15. Orphan designation status: Positive.
First published: 2009-06-09. Last updated: 2013-03-20.

Intended use: Prevention of ischaemia/reperfusion injury associated with solid organ transplantation

Source: https://www.ema.europa.eu/en/medicines/human/orphan-designations/eu-3-09-638